EMA orphan designation EU/3/10/825
Active substance: ovine anti-colchicine polyclonal antibody fragments
Designated 2010-12-17. Orphan designation status: Withdrawn.
First published: 2011-01-20. Last updated: 2026-05-26.

Intended use: Treatment of colchicine poisoning

Source: https://www.ema.europa.eu/en/medicines/human/orphan-designations/eu-3-10-825